EMA orphan designation EU/3/11/882
Active substance: Fresolimumab
Designated 2011-08-05. Orphan designation status: Withdrawn.
First published: 2011-08-16. Last updated: 2016-01-19.

Intended use: Treatment of focal segmental glomerulosclerosis

Source: https://www.ema.europa.eu/en/medicines/human/orphan-designations/eu-3-11-882